EMA orphan designation EU/3/12/1025: Myalepta
Active substance: Metreleptin
Designated 2012-07-17. Orphan designation status: Positive. EMA product number: EMEA/H/C/004218.
First published: 2012-08-31. Last updated: 2024-10-22.

Intended use: Treatment of Berardinelli-Seip syndrome

Source: https://www.ema.europa.eu/en/medicines/human/orphan-designations/eu-3-12-1025